EMA orphan designation EU/3/11/903
Active substance: genetically modified Lactococcus lactis bacteria containing the human trefoil factor 1 gene
Designated 2011-09-27. Orphan designation status: Positive.
First published: 2011-10-17. Last updated: 2016-03-29.

Intended use: Prevention of oral mucositis in head and neck cancer patients undergoing radiation therapy

Source: https://www.ema.europa.eu/en/medicines/human/orphan-designations/eu-3-11-903